EMA orphan designation EU/3/13/1223
Active substance: Inecalcitol
Designated 2014-01-16. Orphan designation status: Positive.
First published: 2014-03-21. Last updated: 2014-03-21.

Intended use: Treatment of chronic lymphocytic leukaemia / small lymphocytic lymphoma

Source: https://www.ema.europa.eu/en/medicines/human/orphan-designations/eu-3-13-1223